EMA orphan designation EU/3/13/1230
Designated 2014-01-16. Orphan designation status: Withdrawn.
First published: 2014-03-24. Last updated: 2024-04-11.

Intended use: Treatment of acute myeloid leukaemia

Source: https://www.ema.europa.eu/en/medicines/human/orphan-designations/eu-3-13-1230